EMA orphan designation EU/3/17/1925
Active substance: Humanised monoclonal antibody targeting B-cell maturation antigen conjugated with maleimidocaproyl monomethyl auristatin F
Designated 2017-10-16. Orphan designation status: Withdrawn.
First published: 2018-01-08. Last updated: 2025-07-17.

Intended use: Treatment of multiple myeloma

Source: https://www.ema.europa.eu/en/medicines/human/orphan-designations/eu-3-17-1925